EMA orphan designation EU/3/06/421
Active substance: forodesine hydrochloride
Designated 2006-12-18. Orphan designation status: Positive.
First published: 2007-12-13. Last updated: 2019-05-21.

Intended use: Treatment of acute lymphoblastic leukaemia

Source: https://www.ema.europa.eu/en/medicines/human/orphan-designations/eu-3-06-421